EMA orphan designation EU/3/12/1028: Bylvay
Designated 2012-07-17. Orphan designation status: Positive. EMA product number: EMEA/H/C/004691.
First published: 2012-08-30. Last updated: 2024-10-11.

Intended use: Treatment of progressive familial intrahepatic cholestasis

Source: https://www.ema.europa.eu/en/medicines/human/orphan-designations/eu-3-12-1028